EMA orphan designation EU/3/08/590
Active substance: 2-[[3-({4-[(5-{2-[(3-Fluorophenyl)amino]-2-oxoethyl}-1H-pyrazol-3-yl)amino]-quinazolin-7-yl}oxy)propyl](ethyl)amino]ethyl dihydrogen phosphate trihydrate
Designated 2008-12-05. Orphan designation status: Withdrawn.
First published: 2009-04-24. Last updated: 2019-08-30.

Intended use: Treatment of acute myeloid leukaemia

Source: https://www.ema.europa.eu/en/medicines/human/orphan-designations/eu-3-08-590